EMA orphan designation EU/3/15/1562
Active substance: staphylococcus aureus protein A
Designated 2015-10-09. Orphan designation status: Positive.
First published: 2015-11-18. Last updated: 2018-06-12.

Intended use: Treatment of immune thrombocytopenia

Source: https://www.ema.europa.eu/en/medicines/human/orphan-designations/eu-3-15-1562